EMA orphan designation EU/3/20/2359
Active substance: adeno-associated viral vector serotype 9 expressing codon-optimized human GBA gene
Designated 2020-11-13. Orphan designation status: Positive.
First published: 2021-02-15.

Intended use: Treatment of frontotemporal dementia

Source: https://www.ema.europa.eu/en/medicines/human/orphan-designations/eu-3-20-2359